EMA orphan designation EU/3/23/2759
Active substance: crisdesalazine
Designated 2023-02-15. Orphan designation status: Positive.
First published: 2024-01-19.

Intended use: Treatment of amyotrophic lateral sclerosis

Source: https://www.ema.europa.eu/en/medicines/human/orphan-designations/eu-3-23-2759